EMA orphan designation EU/3/18/2128
Active substance: Mercaptamine-pantetheine disulfide
Designated 2019-01-11. Orphan designation status: Positive.
First published: 2019-03-27.

Intended use: Treatment of Rett syndrome

Source: https://www.ema.europa.eu/en/medicines/human/orphan-designations/eu-3-18-2128